EMA orphan designation EU/3/22/2659
Active substance: Parsaclisib
Designated 2022-07-18. Orphan designation status: Positive.
First published: 2023-01-23.

Intended use: Treatment of autoimmune haemolytic anaemia

Source: https://www.ema.europa.eu/en/medicines/human/orphan-designations/eu-3-22-2659